EMA orphan designation EU/3/25/3052
Active substance: Parahydroxybenzoic acid
Designated 2025-04-16. Orphan designation status: Positive.
First published: 2025-06-10.

Intended use: Treatment of primary ubiquinone deficiency

Source: https://www.ema.europa.eu/en/medicines/human/orphan-designations/eu-3-25-3052